EMA orphan designation EU/3/16/1617
Active substance: venetoclax
Designated 2016-02-17. Orphan designation status: Withdrawn.
First published: 2016-04-07. Last updated: 2019-04-12.

Intended use: Treatment of acute myeloid leukaemia

Source: https://www.ema.europa.eu/en/medicines/human/orphan-designations/eu-3-16-1617